EMA orphan designation EU/3/21/2436
Active substance: trehalose
Designated 2021-05-20. Orphan designation status: Positive.
First published: 2022-03-18.

Intended use: Treatment of amyotrophic lateral sclerosis

Source: https://www.ema.europa.eu/en/medicines/human/orphan-designations/eu-3-21-2436